EMA orphan designation EU/3/21/2520
Active substance: Fingolimod
Designated 2021-11-12. Orphan designation status: Positive.
First published: 2022-06-08. Last updated: 2022-11-11.

Intended use: Treatment of adrenoleukodystrophy

Source: https://www.ema.europa.eu/en/medicines/human/orphan-designations/eu-3-21-2520